EMA orphan designation EU/3/18/1985
Active substance: rusalatide acetate
Designated 2018-02-22. Orphan designation status: Positive.
First published: 2018-04-11. Last updated: 2020-05-07.

Intended use: Treatment of acute radiation syndrome

Source: https://www.ema.europa.eu/en/medicines/human/orphan-designations/eu-3-18-1985